EMA orphan designation EU/3/22/2722
Active substance: adeno-associated virus serotype 5 vector encoding C1-esterase inhibitor
Designated 2022-11-10. Orphan designation status: Withdrawn.
First published: 2023-01-23. Last updated: 2025-02-20.

Intended use: Treatment of hereditary angioedema

Source: https://www.ema.europa.eu/en/medicines/human/orphan-designations/eu-3-22-2722